EMA orphan designation EU/3/12/1068
Active substance: Melarsoprol
Designated 2012-11-08. Orphan designation status: Positive.
First published: 2012-12-13. Last updated: 2012-12-13.

Intended use: Treatment of African trypanosomiasis

Source: https://www.ema.europa.eu/en/medicines/human/orphan-designations/eu-3-12-1068